EMA orphan designation EU/3/11/907
Active substance: Kifunensine
Designated 2011-09-27. Orphan designation status: Withdrawn.
First published: 2011-10-17. Last updated: 2014-06-19.

Intended use: Treatment of delta sarcoglycanopathy

Source: https://www.ema.europa.eu/en/medicines/human/orphan-designations/eu-3-11-907